EMA orphan designation EU/3/23/2843
Active substance: adeno-associated viral vector serotype 9 containing the human NPC1 gene
Designated 2023-10-13. Orphan designation status: Positive.
First published: 2024-01-24.

Intended use: Treatment of Niemann-Pick's disease, type C

Source: https://www.ema.europa.eu/en/medicines/human/orphan-designations/eu-3-23-2843